EMA orphan designation EU/3/22/2646
Active substance: mRNA encoding modified human ornithine transcarbamylase
Designated 2022-07-18. Orphan designation status: Positive.
First published: 2023-01-23.

Intended use: Treatment of ornithine transcarbamylase deficiency

Source: https://www.ema.europa.eu/en/medicines/human/orphan-designations/eu-3-22-2646